EMA orphan designation EU/3/17/1940
Active substance: concizumab
Designated 2017-10-12. Orphan designation status: Withdrawn.
First published: 2018-01-15. Last updated: 2022-12-14.

Intended use: Treatment of haemophilia B

Source: https://www.ema.europa.eu/en/medicines/human/orphan-designations/eu-3-17-1940